EMA orphan designation EU/3/21/2489
Active substance: cemdisiran
Designated 2021-08-20. Orphan designation status: Positive.
First published: 2022-03-01. Last updated: 2024-10-31.

Intended use: Treatment of primary IgA nephropathy

Source: https://www.ema.europa.eu/en/medicines/human/orphan-designations/eu-3-21-2489